EMA orphan designation EU/3/25/3194
Active substance: saracatinib
Designated 2026-01-09. Orphan designation status: Positive.
First published: 2026-03-25.

Intended use: Treatment of fibrodysplasia ossificans progressiva

Source: https://www.ema.europa.eu/en/medicines/human/orphan-designations/eu-3-25-3194